EMA orphan designation EU/3/18/2071
Active substance: 6-(2-hydroxy-2-methylpropoxy)-4-(6-(6-((6-methoxypyridin-3-yl)methyl)-3,6-diazabicyclo[3.1.1]heptan-3-yl)pyridin-3-yl)pyrazolo[1,5-a]pyridine-3-carbonitrile
Designated 2018-10-26. Orphan designation status: Withdrawn.
First published: 2019-01-11. Last updated: 2020-01-16.

Intended use: Treatment of medullary thyroid carcinoma

Source: https://www.ema.europa.eu/en/medicines/human/orphan-designations/eu-3-18-2071